EMA orphan designation EU/3/13/1227
Active substance: adenovirus-specific T-cells derived from allogeneic donor leukocytes, expanded ex vivo
Designated 2014-01-16. Orphan designation status: Withdrawn.
First published: 2014-03-21. Last updated: 2020-02-12.

Intended use: Treatment of adenovirus infection in allogeneic haematopoietic stem cell transplant recipients

Source: https://www.ema.europa.eu/en/medicines/human/orphan-designations/eu-3-13-1227